EMA orphan designation EU/3/12/1048
Active substance: mixture of two allogeneic human pancreatic cancer cell lines stably transduced with a retroviral vector encoding the murine alpha-(1,3)-galactosyltransferase gene
Designated 2012-10-10. Orphan designation status: Withdrawn.
First published: 2012-11-16. Last updated: 2018-01-23.

Intended use: Treatment of pancreatic cancer

Source: https://www.ema.europa.eu/en/medicines/human/orphan-designations/eu-3-12-1048